EMA orphan designation EU/3/12/1023: Myalepta
Active substance: Metreleptin
Designated 2012-07-17. Orphan designation status: Positive. EMA product number: EMEA/H/C/004218.
First published: 2012-08-31. Last updated: 2024-10-22.

Intended use: Treatment of Barraquer-Simons syndrome

Source: https://www.ema.europa.eu/en/medicines/human/orphan-designations/eu-3-12-1023